EMA orphan designation EU/3/11/875
Active substance: Metronidazole
Designated 2011-06-21. Orphan designation status: Positive.
First published: 2011-07-12. Last updated: 2016-08-15.

Intended use: Treatment of pouchitis

Source: https://www.ema.europa.eu/en/medicines/human/orphan-designations/eu-3-11-875